EMA orphan designation EU/3/14/1337
Active substance: acamprosate calcium
Designated 2014-10-15. Orphan designation status: Positive.
First published: 2014-11-12. Last updated: 2022-11-03.

Intended use: Treatment of fragile X syndrome

Source: https://www.ema.europa.eu/en/medicines/human/orphan-designations/eu-3-14-1337